EMA orphan designation EU/3/21/2401
Designated 2021-02-19. Orphan designation status: Withdrawn.
First published: 2022-02-22. Last updated: 2026-05-26.

Intended use: Treatment of paroxysmal nocturnal haemoglobinuria

Source: https://www.ema.europa.eu/en/medicines/human/orphan-designations/eu-3-21-2401